EMA orphan designation EU/3/05/332
Active substance: 1,2-Bis(methylsulphonyl)-1-(2-chloroethyl)-2-[(methylamino)carbonyl]hydrazine
Designated 2005-12-14. Orphan designation status: Positive.
First published: 2006-02-09. Last updated: 2006-02-09.

Intended use: Treatment of acute myeloid leukaemia

Source: https://www.ema.europa.eu/en/medicines/human/orphan-designations/eu-3-05-332